EMA orphan designation EU/3/25/3136
Active substance: ozisiran
Designated 2025-10-20. Orphan designation status: Positive.
First published: 2026-04-14.

Intended use: Treatment of Glanzmann thrombasthenia

Source: https://www.ema.europa.eu/en/medicines/human/orphan-designations/eu-3-25-3136